EMA orphan designation EU/3/16/1657
Active substance: (R)-6-(2-fluorophenyl)-N-(3-(2-((2-methoxyethyl)amino)ethyl)phenyl)-5,6-dihydrobenzo[h]quinazolin-2-amine dihydrochloride (derazantinib)
Designated 2016-05-30. Orphan designation status: Positive.
First published: 2016-06-28. Last updated: 2019-05-13.

Intended use: Treatment of biliary tract cancer

Source: https://www.ema.europa.eu/en/medicines/human/orphan-designations/eu-3-16-1657